EMA orphan designation EU/3/20/2248
Active substance: Adeno-associated viral vector serotype S3 encoding human alpha-galactosidase A cDNA
Designated 2020-02-28. Orphan designation status: Positive.
First published: 2020-05-05.

Intended use: Treatment of Fabry disease

Source: https://www.ema.europa.eu/en/medicines/human/orphan-designations/eu-3-20-2248